EMA orphan designation EU/3/19/2245
Active substance: setmelanotide
Designated 2020-01-09. Orphan designation status: Positive.
First published: 2020-05-20. Last updated: 2022-07-01.

Intended use: Treatment of Alström syndrome

Source: https://www.ema.europa.eu/en/medicines/human/orphan-designations/eu-3-19-2245